EMA orphan designation EU/3/24/2896
Active substance: human IgG1 monoclonal antibody targeting amyloid transthyretin
Designated 2024-02-19. Orphan designation status: Positive.
First published: 2024-04-11.

Intended use: Treatment of ATTR amyloidosis

Source: https://www.ema.europa.eu/en/medicines/human/orphan-designations/eu-3-24-2896